EMA orphan designation EU/3/14/1325
Active substance: obinutuzumab
Designated 2014-08-22. Orphan designation status: Positive.
First published: 2014-10-02. Last updated: 2015-01-01.

Intended use: Treatment of diffuse large B-cell lymphoma

Source: https://www.ema.europa.eu/en/medicines/human/orphan-designations/eu-3-14-1325-0